EMA orphan designation EU/3/18/2078
Active substance: Peptides YMFPNAPYL, SGQAYMFPNAPYLPSCLES, RSDELVRHHNMHQRNMTKL and PGCNKRYFKLSHLQMHSRKHTG
Designated 2018-10-26. Orphan designation status: Positive.
First published: 2019-01-10.

Intended use: Treatment of multiple myeloma

Source: https://www.ema.europa.eu/en/medicines/human/orphan-designations/eu-3-18-2078